EMA orphan designation EU/3/10/822: Fanskya
Active substance: Lentiviral vector carrying the Fanconi anaemia-A (FANCA) gene
Designated 2010-12-17. Orphan designation status: Positive. EMA product number: EMEA/H/C/005537.
First published: 2011-01-20. Last updated: 2023-09-11.

Intended use: Treatment of Fanconi anaemia type A

Source: https://www.ema.europa.eu/en/medicines/human/orphan-designations/eu-3-10-822